EMA orphan designation EU/3/16/1821
Active substance: leuprorelin acetate
Designated 2017-01-12. Orphan designation status: Positive.
First published: 2017-07-11. Last updated: 2017-07-11.

Intended use: Treatment of congenital hypogonadotropic hypogonadism

Source: https://www.ema.europa.eu/en/medicines/human/orphan-designations/eu-3-16-1821